EMA orphan designation EU/3/14/1361
Active substance: 1-(6-benzothiazolylsulfonyl)-5-chloro-1H-indole-2-butanoic acid (lanifibranor)
Designated 2014-11-19. Orphan designation status: Withdrawn.
First published: 2015-01-15. Last updated: 2020-12-14.

Intended use: Treatment of systemic sclerosis

Source: https://www.ema.europa.eu/en/medicines/human/orphan-designations/eu-3-14-1361